EMA orphan designation EU/3/16/1663
Active substance: pentosan polysulfate sodium
Designated 2016-05-30. Orphan designation status: Positive.
First published: 2016-06-22. Last updated: 2022-05-16.

Intended use: Treatment of interstitial cystitis

Source: https://www.ema.europa.eu/en/medicines/human/orphan-designations/eu-3-16-1663